EMA orphan designation EU/3/05/300
Active substance: nemorubicin hydrochloride
Designated 2005-07-27. Orphan designation status: Positive.
First published: 2005-10-27. Last updated: 2005-10-27.

Intended use: Treatment of hepatocellular carcinoma

Source: https://www.ema.europa.eu/en/medicines/human/orphan-designations/eu-3-05-300